EMA orphan designation EU/3/03/165
Active substance: nolatrexed
Designated 2003-10-02. Orphan designation status: Withdrawn.
First published: 2007-02-23. Last updated: 2007-02-23.

Intended use: Treatment of hepatocellular carcinoma

Source: https://www.ema.europa.eu/en/medicines/human/orphan-designations/eu-3-03-165